EMA orphan designation EU/3/24/2904
Active substance: Mibavademab
Designated 2024-03-21. Orphan designation status: Positive.
First published: 2024-07-23.

Intended use: Treatment of Lawrence syndrome

Source: https://www.ema.europa.eu/en/medicines/human/orphan-designations/eu-3-24-2904